EMA orphan designation EU/3/10/758
Active substance: Pomalidomide
Designated 2010-07-27. Orphan designation status: Withdrawn.
First published: 2010-08-06. Last updated: 2017-02-10.

Intended use: Treatment of post-polycythaemia vera myelofibrosis

Source: https://www.ema.europa.eu/en/medicines/human/orphan-designations/eu-3-10-758